EMA orphan designation EU/3/21/2490
Active substance: recombinant human apolipoprotein A-I
Designated 2021-08-20. Orphan designation status: Positive.
First published: 2022-03-03.

Intended use: Treatment of lecithin-cholesterol acyltransferase deficiency

Source: https://www.ema.europa.eu/en/medicines/human/orphan-designations/eu-3-21-2490